EMA orphan designation EU/3/23/2874
Active substance: (4R)-3-(4-fluoro-2-hydroxyphenyl)-4-methyl-4,5-dihydro-1H-pyrazole-1-carboximidamide hydrochloride
Designated 2024-01-12. Orphan designation status: Withdrawn.
First published: 2024-04-11.

Intended use: Treatment of systemic sclerosis

Source: https://www.ema.europa.eu/en/medicines/human/orphan-designations/eu-3-23-2874